EMA orphan designation EU/3/20/2259
Active substance: 2-hydroxy-N,N,N-trimethylethan-1-aminium (Z)-4-(5-((3-benzyl-4-oxo-2-thioxothiazolidin-5-ylidene)methyl)furan-2-yl)benzoate
Designated 2020-03-24. Orphan designation status: Withdrawn.
First published: 2020-08-12. Last updated: 2023-02-16.

Intended use: Treatment of pancreatic cancer

Source: https://www.ema.europa.eu/en/medicines/human/orphan-designations/eu-3-20-2259